EMA orphan designation EU/3/05/340
Active substance: imatinib mesilate
Designated 2005-12-23. Orphan designation status: Expired.
First published: 2007-02-28. Last updated: 2015-02-09.

Intended use: Treatment of myelodysplastic / myeloproliferative diseases

Source: https://www.ema.europa.eu/en/medicines/human/orphan-designations/eu-3-05-340